EMA orphan designation EU/3/15/1511
Active substance: Human plasminogen
Designated 2015-07-28. Orphan designation status: Positive.
First published: 2015-08-18. Last updated: 2022-01-06.

Intended use: Treatment of plasminogen deficiency

Source: https://www.ema.europa.eu/en/medicines/human/orphan-designations/eu-3-15-1511